EMA orphan designation EU/3/11/904
Active substance: heterologous human adult liver-derived stem cells
Designated 2011-09-27. Orphan designation status: Positive.
First published: 2011-10-17. Last updated: 2021-08-26.

Intended use: Treatment of ornithine transcarbamylase deficiency

Source: https://www.ema.europa.eu/en/medicines/human/orphan-designations/eu-3-11-904